EMA orphan designation EU/3/21/2466
Active substance: Lutetium (177Lu) omburtamab barzuxetan
Designated 2021-07-19. Orphan designation status: Positive.
First published: 2021-11-12.

Intended use: Treatment of medulloblastoma

Source: https://www.ema.europa.eu/en/medicines/human/orphan-designations/eu-3-21-2466